EMA orphan designation EU/3/06/364
Active substance: sorafenib tosilate
Designated 2006-04-11. Orphan designation status: Expired.
First published: 2009-09-23. Last updated: 2018-01-31.

Intended use: Treatment of hepatocellular carcinoma

Source: https://www.ema.europa.eu/en/medicines/human/orphan-designations/eu-3-06-364